EMA orphan designation EU/3/19/2171
Active substance: 5'-cEtG-sp-cEt5MeU-sp-cEt5MeU-sp-dT-sp-dA-sp-dT-sp-dT-sp-dA-sp-dT-sp-dA-sp-dG-sp-dG-sp-dG-sp-cEt5MeC-sp-cEt5MeU-sp-cEt5MeU-3' (also known as DYN101)
Designated 2019-06-28. Orphan designation status: Positive.
First published: 2019-10-03.

Intended use: Treatment of centronuclear myopathies

Source: https://www.ema.europa.eu/en/medicines/human/orphan-designations/eu-3-19-2171